EMA orphan designation EU/3/21/2518
Active substance: paclitaxel; polyoligo(ethylene glycol)methacrylate-co-poly(vinylbenzyldithiodibutyric acid-gemcitabine)
Designated 2021-11-12. Orphan designation status: Positive.
First published: 2022-06-08.

Intended use: Treatment of pancreatic cancer

Source: https://www.ema.europa.eu/en/medicines/human/orphan-designations/eu-3-21-2518